EMA orphan designation EU/3/15/1505
Active substance: obinutuzumab
Designated 2015-06-19. Orphan designation status: Withdrawn.
First published: 2015-07-27. Last updated: 2016-10-20.

Intended use: Treatment of marginal zone lymphoma

Source: https://www.ema.europa.eu/en/medicines/human/orphan-designations/eu-3-15-1505